EMA orphan designation EU/3/21/2532
Active substance: garadacimab
Designated 2021-12-10. Orphan designation status: Withdrawn.
First published: 2022-04-13. Last updated: 2024-12-13.

Intended use: Treatment of hereditary angioedema

Source: https://www.ema.europa.eu/en/medicines/human/orphan-designations/eu-3-21-2532